EMA orphan designation EU/3/14/1310
Designated 2014-08-22. Orphan designation status: Positive.
First published: 2014-09-30. Last updated: 2023-07-18.

Intended use: Treatment of Fabry disease

Source: https://www.ema.europa.eu/en/medicines/human/orphan-designations/eu-3-14-1310